EMA orphan designation EU/3/01/022
Active substance: laronidase
Designated 2001-02-15. Orphan designation status: Expired.
First published: 2009-06-15. Last updated: 2013-07-08.

Intended use: Treatment of mucopolysaccharidosis type I

Source: https://www.ema.europa.eu/en/medicines/human/orphan-designations/eu-3-01-022